EMA orphan designation EU/3/01/020
Active substance: Ibuprofen
Designated 2001-02-15. Orphan designation status: Expired.
First published: 2009-05-15. Last updated: 2015-03-06.

Intended use: Treatment of patent ductus arteriosus

Source: https://www.ema.europa.eu/en/medicines/human/orphan-designations/eu-3-01-020